EMA orphan designation EU/3/21/2502
Active substance: Humanised IgG1 monoclonal antibody against SEZ6 linked to N-acetyl-calicheamicin
Designated 2021-10-15. Orphan designation status: Withdrawn.
First published: 2022-01-20. Last updated: 2023-07-31.

Intended use: Treatment of small cell lung cancer

Source: https://www.ema.europa.eu/en/medicines/human/orphan-designations/eu-3-21-2502